EMA orphan designation EU/3/20/2389
Active substance: Cyclo-L-glycyl-L-2-allylproline
Designated 2021-01-06. Orphan designation status: Positive.
First published: 2021-05-18. Last updated: 2024-10-22.

Intended use: Treatment of Angelman syndrome

Source: https://www.ema.europa.eu/en/medicines/human/orphan-designations/eu-3-20-2389